EMA orphan designation EU/3/25/3139
Active substance: bevantolol hydrochloride
Designated 2025-10-20. Orphan designation status: Positive.
First published: 2026-04-14.

Intended use: Treatment of Huntington's disease

Source: https://www.ema.europa.eu/en/medicines/human/orphan-designations/eu-3-25-3139